EMA orphan designation EU/3/17/1887
Active substance: synthetic glucagon analogue modified to contain 7 amino acid substitutions
Designated 2017-06-20. Orphan designation status: Positive.
First published: 2017-07-17. Last updated: 2020-09-25.

Intended use: Treatment of congenital hyperinsulinism

Source: https://www.ema.europa.eu/en/medicines/human/orphan-designations/eu-3-17-1887